EMA orphan designation EU/3/22/2607
Active substance: adeno-associated virus serotype C102 containing the human GLA gene
Designated 2022-04-13. Orphan designation status: Withdrawn.
First published: 2022-07-19. Last updated: 2025-06-30.

Intended use: Treatment of Fabry disease

Source: https://www.ema.europa.eu/en/medicines/human/orphan-designations/eu-3-22-2607